EMA orphan designation EU/3/21/2491
Active substance: Adeno-associated viral vector serotype S3 containing codon-optimised expression cassette encoding human beta-glucocerebrosidase variant
Designated 2021-08-20. Orphan designation status: Positive.
First published: 2022-03-01.

Intended use: Treatment of Gaucher disease

Source: https://www.ema.europa.eu/en/medicines/human/orphan-designations/eu-3-21-2491